EMA orphan designation EU/3/18/2081
Active substance: 3-(3-(3,5-Dimethyl-1H-pyrazol-4-yl)propoxy)-4-fluorobenzoic acid
Designated 2018-11-19. Orphan designation status: Withdrawn.
First published: 2019-02-19. Last updated: 2025-01-15.

Intended use: Treatment of ATTR amyloidosis

Source: https://www.ema.europa.eu/en/medicines/human/orphan-designations/eu-3-18-2081